EMA orphan designation EU/3/26/3226
Active substance: adeno-associated virus serotype SLB101 containing the human microdystrophin gene
Designated 2026-04-20. Orphan designation status: Positive.
First published: 2026-04-22.

Intended use: Treatment of Duchenne muscular dystrophy

Source: https://www.ema.europa.eu/en/medicines/human/orphan-designations/eu-3-26-3226